EMA orphan designation EU/3/22/2670
Active substance: (R)-3-(2,3-dihydroxypropyl)-6-fluoro-5-(2-fluoro-4-iodophenylamino)-8-methylpyrido[2,3-d]pyrimidine-4,7(3H,8H)-dione
Designated 2022-07-18. Orphan designation status: Positive.
First published: 2023-01-23.

Intended use: Treatment of familial adenomatous polyposis (FAP)

Source: https://www.ema.europa.eu/en/medicines/human/orphan-designations/eu-3-22-2670